EMA orphan designation EU/3/10/797
Active substance: recombinant humanised monoclonal antibody to human Nogo-A protein of the IgG1/kappa class
Designated 2010-10-01. Orphan designation status: Withdrawn.
First published: 2010-10-14. Last updated: 2016-01-19.

Intended use: Treatment of amyotrophic lateral sclerosis

Source: https://www.ema.europa.eu/en/medicines/human/orphan-designations/eu-3-10-797